EMA orphan designation EU/3/06/415
Active substance: ciclosporin
Designated 2006-11-03. Orphan designation status: Withdrawn.
First published: 2009-04-02. Last updated: 2010-03-15.

Intended use: Prevention of rejection for corneal transplant

Source: https://www.ema.europa.eu/en/medicines/human/orphan-designations/eu-3-06-415